EMA orphan designation EU/3/21/2505
Active substance: allogeneic retinal pigment epithelial cells genetically modified with a non-viral vector to express human alpha-L-iduronidase
Designated 2021-10-15. Orphan designation status: Withdrawn.
First published: 2022-01-20. Last updated: 2022-12-20.

Intended use: Treatment of mucopolysaccharidosis type I

Source: https://www.ema.europa.eu/en/medicines/human/orphan-designations/eu-3-21-2505